EMA orphan designation EU/3/03/166
Active substance: Eculizumab
Designated 2003-10-17. Orphan designation status: Expired.
First published: 2009-09-21. Last updated: 2019-11-20.

Intended use: Treatment of paroxysmal nocturnal haemoglobinuria

Source: https://www.ema.europa.eu/en/medicines/human/orphan-designations/eu-3-03-166